EMA orphan designation EU/3/22/2695
Active substance: Epeleuton
Designated 2022-10-11. Orphan designation status: Positive.
First published: 2023-01-24.

Intended use: Treatment of sickle cell disease

Source: https://www.ema.europa.eu/en/medicines/human/orphan-designations/eu-3-22-2695